EMA orphan designation EU/3/12/1062: Qarziba (previously Dinutuximab beta EUSA and Dinutuximab beta Apeiron)
Active substance: chimeric monoclonal antibody against GD2
Designated 2012-11-08. Orphan designation status: Positive. EMA product number: EMEA/H/C/003918.
First published: 2012-12-13. Last updated: 2021-08-26.

Intended use: Treatment of neuroblastoma

Source: https://www.ema.europa.eu/en/medicines/human/orphan-designations/eu-3-12-1062